EMA orphan designation EU/3/11/915
Active substance: 1-(4-{4-Amino-7-[1-(2-hydroxyethyl)-1H-pyrazol-4-yl]thieno[3,2-c]pyridin-3-yl}phenyl)-3-(3-fluorophenyl)urea
Designated 2011-10-27. Orphan designation status: Withdrawn.
First published: 2011-11-23. Last updated: 2015-06-08.

Intended use: Treatment of acute myeloid leukaemia

Source: https://www.ema.europa.eu/en/medicines/human/orphan-designations/eu-3-11-915